EMA orphan designation EU/3/24/2953
Active substance: cannabidiol acid methyl ester
Designated 2024-07-25. Orphan designation status: Positive.
First published: 2024-09-13.

Intended use: Treatment of Prader-Willi syndrome

Source: https://www.ema.europa.eu/en/medicines/human/orphan-designations/eu-3-24-2953